EMA orphan designation EU/3/14/1318
Active substance: ulinastatin
Designated 2014-08-22. Orphan designation status: Positive.
First published: 2014-10-01. Last updated: 2014-10-01.

Intended use: Treatment of acute pancreatitis

Source: https://www.ema.europa.eu/en/medicines/human/orphan-designations/eu-3-14-1318